EMA orphan designation EU/3/14/1331: Reblozyl
Active substance: recombinant fusion protein consisting of a modified form of the extracellular domain of human activin receptor IIB linked to the human IgG1 Fc domain (luspatercept)
Designated 2014-08-22. Orphan designation status: Positive. EMA product number: EMEA/H/C/004444.
First published: 2014-09-29. Last updated: 2021-02-18.

Intended use: Treatment of myelodysplastic syndromes

Source: https://www.ema.europa.eu/en/medicines/human/orphan-designations/eu-3-14-1331